EMA orphan designation EU/3/01/077
Active substance: [Gly2]-recombinant human glucagon-like peptide
Designated 2001-12-12. Orphan designation status: Positive.
First published: 2009-05-15. Last updated: 2022-10-12.

Intended use: Treatment of short bowel syndrome

Source: https://www.ema.europa.eu/en/medicines/human/orphan-designations/eu-3-01-077